EMA orphan designation EU/3/13/1154
Active substance: Fosbretabulin tromethamine
Designated 2013-07-17. Orphan designation status: Withdrawn.
First published: 2013-08-21. Last updated: 2021-09-02.

Intended use: Treatment of ovarian cancer

Source: https://www.ema.europa.eu/en/medicines/human/orphan-designations/eu-3-13-1154